EMA orphan designation EU/3/12/1010
Active substance: ataluren
Designated 2012-07-04. Orphan designation status: Withdrawn.
First published: 2012-07-24. Last updated: 2026-05-18.

Intended use: Treatment of Becker muscular dystrophy

Source: https://www.ema.europa.eu/en/medicines/human/orphan-designations/eu-3-12-1010